EMA orphan designation EU/3/21/2514
Active substance: anti-CD38 IgG4 human monoclonal antibody
Designated 2021-11-12. Orphan designation status: Positive.
First published: 2022-06-07.

Intended use: Treatment of amyotrophic lateral sclerosis

Source: https://www.ema.europa.eu/en/medicines/human/orphan-designations/eu-3-21-2514